EMA orphan designation EU/3/13/1120
Active substance: 4-[2-(6-Methylpyridin-2-yl)-5,6-dihydro-4H-pyrrolo[1,2-b]pyrazol-3-yl]-quinoline-6-carboxamide monohydrate
Designated 2013-04-26. Orphan designation status: Withdrawn.
First published: 2013-05-14. Last updated: 2019-06-17.

Intended use: Treatment of glioma

Source: https://www.ema.europa.eu/en/medicines/human/orphan-designations/eu-3-13-1120